EMA orphan designation EU/3/04/194
Active substance: adeno-associated viral vector expressing lipoprotein lipase (alipogene tiparvovec)
Designated 2004-03-09. Orphan designation status: Withdrawn.
First published: 2007-03-07. Last updated: 2021-05-31.

Intended use: Treatment of lipoprotein-lipase deficiency

Source: https://www.ema.europa.eu/en/medicines/human/orphan-designations/eu-3-04-194